EMA orphan designation EU/3/09/700
Active substance: Vaccinia GM-CSF/TK-deactivated virus
Designated 2009-11-26. Orphan designation status: Positive.
First published: 2009-12-10. Last updated: 2011-11-18.

Intended use: Treatment of hepatocellular carcinoma (pexastimogene devacirepvec)

Source: https://www.ema.europa.eu/en/medicines/human/orphan-designations/eu-3-09-700